EMA orphan designation EU/3/22/2718
Active substance: cannabidiol
Designated 2022-11-11. Orphan designation status: Positive.
First published: 2023-01-23. Last updated: 2023-03-07.

Intended use: Treatment of 22q11.2 deletion syndrome

Source: https://www.ema.europa.eu/en/medicines/human/orphan-designations/eu-3-22-2718